EMA orphan designation EU/3/02/122
Active substance: Etilefrine
Designated 2002-11-13. Orphan designation status: Positive.
First published: 2002-12-13. Last updated: 2013-06-25.

Intended use: Treatment of low-flow priapism

Source: https://www.ema.europa.eu/en/medicines/human/orphan-designations/eu-3-02-122